EMA orphan designation EU/3/02/107
Active substance: proteolytic enzymes enriched in bromelain
Designated 2002-07-30. Orphan designation status: Expired.
First published: 2003-01-08. Last updated: 2023-02-15.

Intended use: Treatment of partial deep dermal and full-thickness burns

Source: https://www.ema.europa.eu/en/medicines/human/orphan-designations/eu-3-02-107